EMA orphan designation EU/3/07/501
Active substance: olaparib
Designated 2007-12-06. Orphan designation status: Withdrawn.
First published: 2008-07-02. Last updated: 2018-05-28.

Intended use: Treatment of ovarian cancer

Source: https://www.ema.europa.eu/en/medicines/human/orphan-designations/eu-3-07-501